EMA orphan designation EU/3/23/2854
Active substance: Humanised IgG1 monoclonal antibody against CLDN6 conjugated to monomethyl auristatin E via a cathepsin hydrolysable dipeptide VC linker
Designated 2023-11-08. Orphan designation status: Positive.
First published: 2024-01-31.

Intended use: Treatment of ovarian cancer

Source: https://www.ema.europa.eu/en/medicines/human/orphan-designations/eu-3-23-2854